EMA orphan designation EU/3/09/708: Palynziq
Active substance: pegylated recombinant phenylalanine ammonia lyase (pegvaliase)
Designated 2010-01-28. Orphan designation status: Positive. EMA product number: EMEA/H/C/004744.
First published: 2010-02-25. Last updated: 2019-05-29.

Intended use: Treatment of hyperphenylalaninaemia

Source: https://www.ema.europa.eu/en/medicines/human/orphan-designations/eu-3-09-708